EMA orphan designation EU/3/20/2274
Active substance: adeno-associated virus vector serotype hu37 encoding human factor VIII
Designated 2020-04-22. Orphan designation status: Withdrawn.
First published: 2020-08-13. Last updated: 2026-05-26.

Intended use: Treatment of haemophilia A

Source: https://www.ema.europa.eu/en/medicines/human/orphan-designations/eu-3-20-2274